EMA orphan designation EU/3/22/2604
Active substance: (2S)‑4‑[2‑methoxyethyl‑[4‑(5,6,7,8‑tetrahydro‑1,8‑naphthyridin‑2‑yl)butyl]amino]‑2‑(quinazolin‑4‑ylamino)butanoic acid
Designated 2022-04-13. Orphan designation status: Withdrawn.
First published: 2022-07-19.

Intended use: Treatment of primary sclerosing cholangitis

Source: https://www.ema.europa.eu/en/medicines/human/orphan-designations/eu-3-22-2604